EMA orphan designation EU/3/23/2872
Active substance: ovine polyclonal fragment antigen-binding against ricin
Designated 2023-12-13. Orphan designation status: Positive.
First published: 2024-02-01.

Intended use: Treatment of ricin poisoning

Source: https://www.ema.europa.eu/en/medicines/human/orphan-designations/eu-3-23-2872